EMA orphan designation EU/3/15/1448
Active substance: 505 amino acid protein, corresponding to amino acids 2-506 of the wild type human histidyl-tRNA synthetase
Designated 2015-02-12. Orphan designation status: Withdrawn.
First published: 2015-04-09. Last updated: 2020-02-12.

Intended use: treatment of facioscapulohumeral muscular dystrophy

Source: https://www.ema.europa.eu/en/medicines/human/orphan-designations/eu-3-15-1448